EMA orphan designation EU/3/17/1967
Active substance: N-[2,6-bis(1-methylethyl)phenyl]-N'-[[1-[4-(dimethylamino)phenyl]cyclopentyl]methyl]urea, hydrochloride salt
Designated 2018-01-17. Orphan designation status: Positive.
First published: 2018-03-13. Last updated: 2019-04-26.

Intended use: Treatment of congenital adrenal hyperplasia

Source: https://www.ema.europa.eu/en/medicines/human/orphan-designations/eu-3-17-1967